EMA orphan designation EU/3/23/2808
Active substance: vonafexor
Designated 2023-07-25. Orphan designation status: Positive.
First published: 2024-02-01.

Intended use: Treatment of Alport syndrome

Source: https://www.ema.europa.eu/en/medicines/human/orphan-designations/eu-3-23-2808